EMA orphan designation EU/3/24/2966
Active substance: bemarituzumab
Designated 2024-08-21. Orphan designation status: Withdrawn.
First published: 2024-09-19. Last updated: 2026-05-18.

Intended use: Treatment of gastric cancer

Source: https://www.ema.europa.eu/en/medicines/human/orphan-designations/eu-3-24-2966